EMA orphan designation EU/3/03/140
Active substance: tobramycin
Designated 2003-03-17. Orphan designation status: Expired.
First published: 2007-03-07. Last updated: 2023-07-31.

Intended use: Treatment of Pseudomonas aeruginosa lung infection in cystic fibrosis

Source: https://www.ema.europa.eu/en/medicines/human/orphan-designations/eu-3-03-140